EMA orphan designation EU/3/21/2571
Designated 2022-01-14. Orphan designation status: Positive.
First published: 2022-06-16.

Intended use: Treatment of Leber’s hereditary optic neuropathy

Source: https://www.ema.europa.eu/en/medicines/human/orphan-designations/eu-3-21-2571